EMA orphan designation EU/3/24/2967
Active substance: adeno-associated virus vector serotype 9 containing the human GCDH gene
Designated 2024-08-21. Orphan designation status: Positive.
First published: 2024-09-19.

Intended use: Treatment of glutaric aciduria

Source: https://www.ema.europa.eu/en/medicines/human/orphan-designations/eu-3-24-2967